EMA orphan designation EU/3/06/416
Active substance: antisense oligonucleotide 5'-d[P-Thio] (CCCTG CTCCC CCCTG GCTCC)-3'
Designated 2006-11-03. Orphan designation status: Positive.
First published: 2009-04-02. Last updated: 2015-03-23.

Intended use: Treatment of acute myeloid leukaemia

Source: https://www.ema.europa.eu/en/medicines/human/orphan-designations/eu-3-06-416